EMA orphan designation EU/3/01/069
Active substance: phenylephrine hydrochloride
Designated 2001-11-20. Orphan designation status: Positive.
First published: 2009-05-15. Last updated: 2009-05-15.

Intended use: Treatment of ileal pouch anal anastomosis (IPAA) related faecal incontinence

Source: https://www.ema.europa.eu/en/medicines/human/orphan-designations/eu-3-01-069